EMA orphan designation EU/3/21/2397: Kimmtrak
Active substance: tebentafusp
Designated 2021-02-19. Orphan designation status: Positive. EMA product number: EMEA/H/C/004929.
First published: 2022-02-22. Last updated: 2022-04-22.

Intended use: Treatment of uveal melanoma

Source: https://www.ema.europa.eu/en/medicines/human/orphan-designations/eu-3-21-2397